EMA orphan designation EU/3/20/2351
Active substance: adeno-associated virus serotype 5 containing the human RDH12 gene
Designated 2020-10-19. Orphan designation status: Positive.
First published: 2021-02-18. Last updated: 2024-10-22.

Intended use: Treatment of RDH12 mutation associated retinal dystrophy

Source: https://www.ema.europa.eu/en/medicines/human/orphan-designations/eu-3-20-2351